EMA orphan designation EU/3/02/087
Active substance: eflornithine hydrochloride
Designated 2002-02-19. Orphan designation status: Withdrawn.
First published: 2005-11-29. Last updated: 2005-11-29.

Intended use: Treatment of familial adenomatous polyposis (FAP)

Source: https://www.ema.europa.eu/en/medicines/human/orphan-designations/eu-3-02-087